EMA orphan designation EU/3/07/447
Active substance: nilotinib hydrochloride monohydrate
Designated 2007-04-13. Orphan designation status: Withdrawn.
First published: 2008-07-29. Last updated: 2015-02-10.

Intended use: Treatment of gastrointestinal stromal tumours

Source: https://www.ema.europa.eu/en/medicines/human/orphan-designations/eu-3-07-447